EMA orphan designation EU/3/14/1395
Active substance: allogeneic ex vivo-generated natural killer cells from CD34+ umbilical cord blood progenitor cells
Designated 2014-12-16. Orphan designation status: Positive.
First published: 2015-02-26. Last updated: 2015-02-26.

Intended use: Treatment of acute myeloid leukaemia

Source: https://www.ema.europa.eu/en/medicines/human/orphan-designations/eu-3-14-1395